EMA orphan designation EU/3/09/618
Active substance: allogeneic ex-vivo-expanded umbilical-cord blood cells
Designated 2009-02-27. Orphan designation status: Withdrawn.
First published: 2009-10-30. Last updated: 2015-05-08.

Intended use: Treatment of acute lymphoblastic leukaemia

Source: https://www.ema.europa.eu/en/medicines/human/orphan-designations/eu-3-09-618